EMA orphan designation EU/3/06/394: Lympreva
Active substance: autologous tumour-derived immunoglobulin idiotype coupled to keyhole limpet hemocyanin
Designated 2006-08-28. Orphan designation status: Positive. EMA product number: EMEA/H/C/002772.
First published: 2009-04-02. Last updated: 2012-06-14.

Intended use: Treatment of follicular lymphoma

Source: https://www.ema.europa.eu/en/medicines/human/orphan-designations/eu-3-06-394